EMA orphan designation EU/3/18/2031
Active substance: argon
Designated 2018-06-27. Orphan designation status: Positive.
First published: 2018-08-22. Last updated: 2018-08-22.

Intended use: Treatment of perinatal asphyxia

Source: https://www.ema.europa.eu/en/medicines/human/orphan-designations/eu-3-18-2031